EMA orphan designation EU/3/19/2193
Active substance: acetazolamide
Designated 2019-08-21. Orphan designation status: Positive.
First published: 2020-01-14.

Intended use: Treatment of periodic paralysis

Source: https://www.ema.europa.eu/en/medicines/human/orphan-designations/eu-3-19-2193